EMA orphan designation EU/3/23/2823
Active substance: 2'-O-(2-methoxyethyl) modified antisense oligonucleotide targeting PLP1 pre-mRNA
Designated 2023-08-16. Orphan designation status: Positive.
First published: 2024-02-02.

Intended use: Treatment of Pelizaeus-Merzbacher disease

Source: https://www.ema.europa.eu/en/medicines/human/orphan-designations/eu-3-23-2823